EMA orphan designation EU/3/19/2159
Active substance: (S)-5-(1-(6-chloro-2-oxo-1,2-dihydroquinolin-3-yl)ethylamino-1-methyl-6-oxo-1,6-dihydropyridine-2-carbonitrile (olutasidenib)
Designated 2019-05-29. Orphan designation status: Positive.
First published: 2019-08-09. Last updated: 2023-01-23.

Intended use: Treatment of acute myeloid leukaemia

Source: https://www.ema.europa.eu/en/medicines/human/orphan-designations/eu-3-19-2159